EMA orphan designation EU/3/14/1355
Active substance: selinexor
Designated 2014-11-19. Orphan designation status: Positive.
First published: 2015-01-15. Last updated: 2021-06-07.

Intended use: Treatment of plasma cell myeloma

Source: https://www.ema.europa.eu/en/medicines/human/orphan-designations/eu-3-14-1355